EMA orphan designation EU/3/12/956
Active substance: human monoclonal antibody against Fas ligand
Designated 2012-02-09. Orphan designation status: Positive.
First published: 2012-03-01. Last updated: 2020-06-11.

Intended use: Treatment of pemphigus

Source: https://www.ema.europa.eu/en/medicines/human/orphan-designations/eu-3-12-956